EMA orphan designation EU/3/19/2157
Active substance: sodium benzoate; sodium phenylacetate
Designated 2019-04-24. Orphan designation status: Positive.
First published: 2019-06-14.

Intended use: Treatment of ornithine transcarbamylase deficiency

Source: https://www.ema.europa.eu/en/medicines/human/orphan-designations/eu-3-19-2157